EMA orphan designation EU/3/16/1696
Active substance: autologous Epstein-Barr virus specific T cells derived from peripheral blood mononuclear cells, expanded ex vivo
Designated 2016-07-14. Orphan designation status: Withdrawn.
First published: 2016-09-06. Last updated: 2020-05-25.

Intended use: Treatment of post-transplantation lymphoproliferative disorders

Source: https://www.ema.europa.eu/en/medicines/human/orphan-designations/eu-3-16-1696